EMA orphan designation EU/3/26/3215
Active substance: atigotatug; nivolumab
Designated 2026-03-25. Orphan designation status: Positive.
First published: 2026-04-22.

Intended use: Treatment of pulmonary neuroendocrine carcinoma

Source: https://www.ema.europa.eu/en/medicines/human/orphan-designations/eu-3-26-3215